EMA orphan designation EU/3/14/1425
Active substance: ceftriaxone
Designated 2015-01-15. Orphan designation status: Positive.
First published: 2015-03-06. Last updated: 2015-03-06.

Intended use: Treatment of spinocerebellar ataxia

Source: https://www.ema.europa.eu/en/medicines/human/orphan-designations/eu-3-14-1425